EMA orphan designation EU/3/20/2343
Active substance: retifanlimab
Designated 2020-10-19. Orphan designation status: Positive.
First published: 2021-02-16.

Intended use: Treatment of anal cancer

Source: https://www.ema.europa.eu/en/medicines/human/orphan-designations/eu-3-20-2343